EMA orphan designation EU/3/22/2712
Active substance: Iodine (124I) evuzamitide
Designated 2022-11-11. Orphan designation status: Positive.
First published: 2023-01-23. Last updated: 2023-07-25.

Intended use: Diagnosis of AL amyloidosis

Source: https://www.ema.europa.eu/en/medicines/human/orphan-designations/eu-3-22-2712